EMA orphan designation EU/3/20/2339: Joenja
Active substance: leniolisib
Designated 2020-10-19. Orphan designation status: Positive. EMA product number: EMEA/H/C/005927.
First published: 2021-05-25. Last updated: 2026-03-27.

Intended use: Treatment of activated phosphoinositide 3-kinase delta syndrome

Source: https://www.ema.europa.eu/en/medicines/human/orphan-designations/eu-3-20-2339